EMA orphan designation EU/3/05/346
Active substance: E. Coli heat-shock protein 70 with bovine retinal S-antigen
Designated 2006-01-24. Orphan designation status: Positive.
First published: 2006-08-08. Last updated: 2006-08-08.

Intended use: Treatment of autoimmune uveitis

Source: https://www.ema.europa.eu/en/medicines/human/orphan-designations/eu-3-05-346